EMA orphan designation EU/3/08/583
Active substance: gadodiamide
Designated 2008-12-03. Orphan designation status: Positive.
First published: 2009-06-29. Last updated: 2009-06-29.

Intended use: Treatment of glioma

Source: https://www.ema.europa.eu/en/medicines/human/orphan-designations/eu-3-08-583